EMA orphan designation EU/3/23/2867
Active substance: Methyl 4-(2-acetamidoethylsulfanyl)-4-oxobutanoate
Designated 2023-12-13. Orphan designation status: Positive.
First published: 2024-02-01.

Intended use: Treatment of Leigh syndrome

Source: https://www.ema.europa.eu/en/medicines/human/orphan-designations/eu-3-23-2867